EMA orphan designation EU/3/18/2092
Active substance: H-Arg-Pro-Lys-Pro-Gln-Gln-Phe-2Thi-Gly-Leu-Met(O2)-NH2-DOTA-213-bismuth
Designated 2018-11-19. Orphan designation status: Positive.
First published: 2019-02-25.

Intended use: Treatment of glioma

Source: https://www.ema.europa.eu/en/medicines/human/orphan-designations/eu-3-18-2092